EMA orphan designation EU/3/24/2978
Active substance: Ivosidenib
Designated 2024-11-10. Orphan designation status: Positive.
First published: 2024-11-20.

Intended use: Treatment of chondrosarcoma

Source: https://www.ema.europa.eu/en/medicines/human/orphan-designations/eu-3-24-2978